EMA orphan designation EU/3/16/1720
Active substance: Fimaporfin
Designated 2016-08-29. Orphan designation status: Positive.
First published: 2016-10-10. Last updated: 2016-10-10.

Intended use: Treatment of cholangiocarcinoma

Source: https://www.ema.europa.eu/en/medicines/human/orphan-designations/eu-3-16-1720